EMA orphan designation EU/3/17/1892
Active substance: Oxymetazoline hydrochloride
Designated 2017-07-17. Orphan designation status: Positive.
First published: 2017-08-15. Last updated: 2017-08-15.

Intended use: Treatment of spinal cord injury

Source: https://www.ema.europa.eu/en/medicines/human/orphan-designations/eu-3-17-1892